EMA orphan designation EU/3/19/2153
Active substance: autologous human bone marrow-derived haematopoietic and mesenchymal stem cells depleted of erythrocytes, monocytes and lymphocytes
Designated 2019-04-24. Orphan designation status: Positive.
First published: 2019-06-14.

Intended use: Treatment of spinal cord injury

Source: https://www.ema.europa.eu/en/medicines/human/orphan-designations/eu-3-19-2153